EMA orphan designation EU/3/22/2723
Active substance: upifitamab rilsodotin
Designated 2022-12-09. Orphan designation status: Withdrawn.
First published: 2023-03-13. Last updated: 2025-03-12.

Intended use: Treatment of ovarian cancer

Source: https://www.ema.europa.eu/en/medicines/human/orphan-designations/eu-3-22-2723